EMA orphan designation EU/3/21/2476
Active substance: 3,5-diiodothyropropionic acid
Designated 2021-07-19. Orphan designation status: Positive.
First published: 2021-11-16.

Intended use: Treatment of Allan-Herndon-Dudley syndrome

Source: https://www.ema.europa.eu/en/medicines/human/orphan-designations/eu-3-21-2476